EMA orphan designation EU/3/18/2064: Aliqopa
Active substance: Copanlisib
Designated 2018-08-24. Orphan designation status: Withdrawn. EMA product number: EMEA/H/C/004334.
First published: 2018-12-19. Last updated: 2026-05-26.

Intended use: Treatment of marginal zone lymphoma

Source: https://www.ema.europa.eu/en/medicines/human/orphan-designations/eu-3-18-2064